EMA orphan designation EU/3/22/2574
Active substance: pyridoxal 5'-phosphate
Designated 2022-02-24. Orphan designation status: Positive.
First published: 2022-04-28.

Intended use: Treatment of pyridoxamine 5'-phosphate oxidase deficiency

Source: https://www.ema.europa.eu/en/medicines/human/orphan-designations/eu-3-22-2574